EMA orphan designation EU/3/17/1917
Active substance: adenoviral vector of serotype 5 modified to contain a chimeric sequence consisting of a minimal urokinase-type plasminogen activator receptor promoter preceded by three Notch-responsive elements, and coated with oligopeptide end-modified poly (beta-amino) esters
Designated 2017-10-16. Orphan designation status: Positive.
First published: 2018-01-09. Last updated: 2018-01-09.

Intended use: Treatment of pancreatic cancer

Source: https://www.ema.europa.eu/en/medicines/human/orphan-designations/eu-3-17-1917